EMA orphan designation EU/3/09/694
Active substance: 6-Thioguanine
Designated 2009-11-26. Orphan designation status: Positive.
First published: 2009-12-10. Last updated: 2013-11-28.

Intended use: Treatment of acute lymphoblastic leukaemia

Source: https://www.ema.europa.eu/en/medicines/human/orphan-designations/eu-3-09-694